EMA orphan designation EU/3/13/1134
Active substance: autologous CD34+ cells transduced with a lentiviral vector containing the human ADA gene
Designated 2013-06-07. Orphan designation status: Positive.
First published: 2013-06-25. Last updated: 2023-04-03.

Intended use: Treatment of adenosine-deaminase-deficient severe combined immunodeficiency

Source: https://www.ema.europa.eu/en/medicines/human/orphan-designations/eu-3-13-1134